EMA orphan designation EU/3/22/2599
Active substance: belzupacap sarotalocan
Designated 2022-04-13. Orphan designation status: Positive.
First published: 2022-07-19. Last updated: 2023-09-20.

Intended use: Treatment of uveal melanoma

Source: https://www.ema.europa.eu/en/medicines/human/orphan-designations/eu-3-22-2599